EMA orphan designation EU/3/07/471: Coagadex
Active substance: human coagulation factor X
Designated 2007-09-14. Orphan designation status: Positive. EMA product number: EMEA/H/C/003855.
First published: 2008-07-29. Last updated: 2019-03-26.

Intended use: Treatment of hereditary factor X deficiency

Source: https://www.ema.europa.eu/en/medicines/human/orphan-designations/eu-3-07-471